EMA orphan designation EU/3/22/2734
Active substance: potassium 2-chloro-3-(1-hydroxy-5,6,7,8-tetrahydronaphthalen-2-yl)-6-oxo-5-phenyl-6,7-dihydrothieno[2,3-b]pyridin-4-olate monohydrate
Designated 2022-12-09. Orphan designation status: Positive.
First published: 2023-03-13.

Intended use: Treatment of adrenoleukodystrophy

Source: https://www.ema.europa.eu/en/medicines/human/orphan-designations/eu-3-22-2734